EMA orphan designation EU/3/03/179
Active substance: recombinant human factor XIII (composed of two A subunits)
Designated 2003-12-12. Orphan designation status: Withdrawn.
First published: 2005-12-12. Last updated: 2017-02-10.

Intended use: Treatment of hereditary factor-XIII deficiency

Source: https://www.ema.europa.eu/en/medicines/human/orphan-designations/eu-3-03-179